EMA orphan designation EU/3/19/2174
Active substance: rasagiline
Designated 2019-06-28. Orphan designation status: Withdrawn.
First published: 2019-10-10. Last updated: 2021-01-12.

Intended use: Treatment of Duchenne muscular dystrophy

Source: https://www.ema.europa.eu/en/medicines/human/orphan-designations/eu-3-19-2174